EMA orphan designation EU/3/23/2840
Active substance: (R)-(3-(2'-cyclopropyl-3-(hydroxymethyl)-[1,1'-biphenyl]-4-yl) pyrrolidin-1-yl)(5-fluoropyridin-2-yl)methanone
Designated 2023-10-13. Orphan designation status: Positive.
First published: 2024-02-01.

Intended use: Treatment of Olmsted syndrome

Source: https://www.ema.europa.eu/en/medicines/human/orphan-designations/eu-3-23-2840